EMA orphan designation EU/3/22/2673
Active substance: nanatinostat; valganciclovir
Designated 2022-08-10. Orphan designation status: Withdrawn.
First published: 2022-12-15. Last updated: 2025-09-04.

Intended use: Treatment of peripheral T-cell lymphoma

Source: https://www.ema.europa.eu/en/medicines/human/orphan-designations/eu-3-22-2673